EMA orphan designation EU/3/03/155
Active substance: murine anti-idiotypic antibody against OC125 antibody against CA125 antigen
Designated 2003-07-09. Orphan designation status: Withdrawn.
First published: 2006-01-04. Last updated: 2014-01-13.

Intended use: Treatment of ovarian cancer

Source: https://www.ema.europa.eu/en/medicines/human/orphan-designations/eu-3-03-155